EMA orphan designation EU/3/13/1181: Jorveza
Active substance: Budesonide
Designated 2013-08-05. Orphan designation status: Positive. EMA product number: EMEA/H/C/004655.
First published: 2013-09-13. Last updated: 2018-01-18.

Intended use: Treatment of eosinophilic oesophagitis

Source: https://www.ema.europa.eu/en/medicines/human/orphan-designations/eu-3-13-1181